EMA orphan designation EU/3/07/441
Active substance: hydrocortisone
Designated 2007-03-20. Orphan designation status: Withdrawn.
First published: 2009-09-21. Last updated: 2025-09-04.

Intended use: Treatment of adrenal insufficiency

Source: https://www.ema.europa.eu/en/medicines/human/orphan-designations/eu-3-07-441